EMA orphan designation EU/3/13/1210: Natpar
Active substance: recombinant human parathyroid hormone
Designated 2014-01-16. Orphan designation status: Positive. EMA product number: EMEA/H/C/003861.
First published: 2014-01-23. Last updated: 2022-10-04.

Intended use: Treatment of hypoparathyroidism

Source: https://www.ema.europa.eu/en/medicines/human/orphan-designations/eu-3-13-1210